EMA orphan designation EU/3/15/1484
Active substance: nitric oxide
Designated 2015-04-24. Orphan designation status: Withdrawn.
First published: 2015-05-22. Last updated: 2019-04-12.

Intended use: Treatment of cystic fibrosis

Source: https://www.ema.europa.eu/en/medicines/human/orphan-designations/eu-3-15-1484